EMA orphan designation EU/3/05/302
Active substance: Extract of Sorghum bicolour leaf, Pterocarpus osun stem, Piper guineense seed and Caryophylli flower
Designated 2005-08-26. Orphan designation status: Positive.
First published: 2005-10-27. Last updated: 2005-10-27.

Intended use: Treatment of sickle cell disease

Source: https://www.ema.europa.eu/en/medicines/human/orphan-designations/eu-3-05-302